EMA orphan designation EU/3/13/1221
Active substance: Humanised monoclonal modified IgG4 antibody with bispecific structure targeting factors IX, IXa, X and Xa (emicizumab)
Designated 2014-01-16. Orphan designation status: Withdrawn.
First published: 2014-01-23. Last updated: 2017-07-17.

Intended use: Treatment of haemophilia A

Source: https://www.ema.europa.eu/en/medicines/human/orphan-designations/eu-3-13-1221